EMA orphan designation EU/3/02/095
Active substance: Iodine (131I) chimeric IgG monoclonal antibody cG250
Designated 2002-03-19. Orphan designation status: Withdrawn.
First published: 2003-01-06. Last updated: 2014-06-18.

Intended use: Treatment of renal-cell carcinoma

Source: https://www.ema.europa.eu/en/medicines/human/orphan-designations/eu-3-02-095